EMA orphan designation EU/3/13/1141
Active substance: synthetic double-stranded siRNA oligonucleotide directed against the keratin 6a N171K mutation
Designated 2013-06-19. Orphan designation status: Positive.
First published: 2013-07-11. Last updated: 2013-07-11.

Intended use: Treatment of pachyonychia congenita

Source: https://www.ema.europa.eu/en/medicines/human/orphan-designations/eu-3-13-1141